EMA orphan designation EU/3/14/1407
Active substance: allogeneic adipose-derived adult mesenchymal stem cells contained in a fibrin-based bioengineered dermis
Designated 2014-12-16. Orphan designation status: Positive.
First published: 2015-02-27. Last updated: 2015-02-27.

Intended use: Treatment of epidermolysis bullosa

Source: https://www.ema.europa.eu/en/medicines/human/orphan-designations/eu-3-14-1407